EMA orphan designation EU/3/21/2538
Active substance: anti-(endothelin-1 receptor subtype A) IgG4 humanised monoclonal antibody
Designated 2021-12-10. Orphan designation status: Positive.
First published: 2022-04-13.

Intended use: Treatment of pulmonary arterial hypertension

Source: https://www.ema.europa.eu/en/medicines/human/orphan-designations/eu-3-21-2538